EMA orphan designation EU/3/10/760: Zejula
Active substance: (3S)-3-{4-[7-(aminocarbonyl)-2H-indazol-2-yl] phenyl} piperidine tosilate monohydrate salt (niraparib)
Designated 2010-08-04. Orphan designation status: Positive. EMA product number: EMEA/H/C/004249.
First published: 2010-08-11. Last updated: 2020-11-06.

Intended use: Treatment of ovarian cancer

Source: https://www.ema.europa.eu/en/medicines/human/orphan-designations/eu-3-10-760